EMA orphan designation EU/3/07/521
Active substance: H-Arg-Leu-Phe-Phe-Tyr-Arg-Lys-Ser-Val-OH, acetate salt& H-Tyr-Leu-Phe-Phe-Tyr-Arg-Lys-Ser-Val-OH, acetate salt
Designated 2007-12-18. Orphan designation status: Positive.
First published: 2008-10-21. Last updated: 2010-03-15.

Intended use: Treatment of TERT positive non-small cell lung cancer in HLA-A2 positive patients

Source: https://www.ema.europa.eu/en/medicines/human/orphan-designations/eu-3-07-521